EMA orphan designation EU/3/15/1469
Active substance: Human reovirus type 3 Dearing strain
Designated 2015-03-19. Orphan designation status: Positive.
First published: 2015-05-11. Last updated: 2015-05-11.

Intended use: Treatment of ovarian cancer

Source: https://www.ema.europa.eu/en/medicines/human/orphan-designations/eu-3-15-1469